EMA orphan designation EU/3/08/567
Active substance: Miltefosine
Designated 2008-09-22. Orphan designation status: Positive.
First published: 2009-04-24. Last updated: 2009-04-24.

Intended use: Treatment of cutaneous T-cell lymphoma

Source: https://www.ema.europa.eu/en/medicines/human/orphan-designations/eu-3-08-567